EMA orphan designation EU/3/26/3197
Active substance: (4Z,7R,8E,10E,12Z,14S,16Z,19Z)-7,14-Dihydroxydocosa-4,8,10,12,16,19-hexaenoic acid
Designated 2026-02-23. Orphan designation status: Positive.
First published: 2026-04-10.

Intended use: Treatment of spinal cord injury

Source: https://www.ema.europa.eu/en/medicines/human/orphan-designations/eu-3-26-3197